EMA orphan designation EU/3/05/333
Active substance: eptacog alfa (activated)
Designated 2005-12-14. Orphan designation status: Withdrawn.
First published: 2009-09-21. Last updated: 2021-09-21.

Intended use: Treatment of diffuse alveolar haemorrhage

Source: https://www.ema.europa.eu/en/medicines/human/orphan-designations/eu-3-05-333